EMA orphan designation EU/3/12/999
Active substance: Letermovir
Designated 2012-06-06. Orphan designation status: Positive.
First published: 2012-07-04. Last updated: 2018-06-28.

Intended use: Treatment of cytomegalovirus disease in patients with impaired cell-mediated immunity

Source: https://www.ema.europa.eu/en/medicines/human/orphan-designations/eu-3-12-999